EMA orphan designation EU/3/06/395
Active substance: aviptadil
Designated 2006-08-28. Orphan designation status: Positive.
First published: 2009-04-02. Last updated: 2009-04-02.

Intended use: Treatment of acute lung Injury

Source: https://www.ema.europa.eu/en/medicines/human/orphan-designations/eu-3-06-395